EMA orphan designation EU/3/21/2519
Active substance: autologous CD34+ enriched cells transduced with a self-inactivating lentiviral vector containing the codon-optimized RPS19 gene
Designated 2021-11-12. Orphan designation status: Positive.
First published: 2022-06-08. Last updated: 2022-11-11.

Intended use: Treatment of Diamond-Blackfan anaemia

Source: https://www.ema.europa.eu/en/medicines/human/orphan-designations/eu-3-21-2519